EMA orphan designation EU/3/24/2993
Active substance: 4-[[(4-Methoxyphenyl)thio]methyl]-N,N-dimethyl-1H-1,2,3-triazole-1-ethanamine
Designated 2024-11-11. Orphan designation status: Positive.
First published: 2024-11-21.

Intended use: Treatment of non-syndromic inherited retinal dystrophies with a rod-dominant phenotype

Source: https://www.ema.europa.eu/en/medicines/human/orphan-designations/eu-3-24-2993